EMA orphan designation EU/3/17/1945: Emcitate
Active substance: tiratricol
Designated 2017-10-12. Orphan designation status: Positive. EMA product number: EMEA/H/C/005220.
First published: 2018-01-15. Last updated: 2024-12-13.

Intended use: Treatment of Allan-Herndon-Dudley syndrome

Source: https://www.ema.europa.eu/en/medicines/human/orphan-designations/eu-3-17-1945